EMA orphan designation EU/3/22/2696
Active substance: velusetrag
Designated 2022-10-11. Orphan designation status: Positive.
First published: 2023-01-24.

Intended use: Treatment of intestinal pseudo-obstruction

Source: https://www.ema.europa.eu/en/medicines/human/orphan-designations/eu-3-22-2696